EMA orphan designation EU/3/05/315
Designated 2005-08-26. Orphan designation status: Withdrawn.
First published: 2008-08-18. Last updated: 2022-11-08.

Intended use: Treatment of progressive myoclonic epilepsies

Source: https://www.ema.europa.eu/en/medicines/human/orphan-designations/eu-3-05-315